EMA orphan designation EU/3/19/2135
Active substance: allogeneic cultured postnatal thymus-derived tissue
Designated 2019-02-26. Orphan designation status: Withdrawn.
First published: 2019-04-09. Last updated: 2024-04-08.

Intended use: Treatment of DiGeorge syndrome

Source: https://www.ema.europa.eu/en/medicines/human/orphan-designations/eu-3-19-2135